EMA orphan designation EU/3/17/1910: Hyftor
Active substance: sirolimus
Designated 2017-08-23. Orphan designation status: Positive. EMA product number: EMEA/H/C/005896.
First published: 2017-10-17. Last updated: 2023-06-09.

Intended use: Treatment of tuberous sclerosis

Source: https://www.ema.europa.eu/en/medicines/human/orphan-designations/eu-3-17-1910